EMA orphan designation EU/3/16/1774
Active substance: allogeneic peripheral blood mononuclear cells incubated ex-vivo with 16, 16-dimethyl prostaglandin E2; dexamethasone
Designated 2016-11-18. Orphan designation status: Withdrawn.
First published: 2016-12-13. Last updated: 2022-08-10.

Intended use: Treatment in haematopoietic stem cell transplantation

Source: https://www.ema.europa.eu/en/medicines/human/orphan-designations/eu-3-16-1774